EMA orphan designation EU/3/15/1502
Active substance: trehalose
Designated 2015-06-19. Orphan designation status: Positive.
First published: 2015-07-27. Last updated: 2020-06-08.

Intended use: Treatment of spinocerebellar ataxia

Source: https://www.ema.europa.eu/en/medicines/human/orphan-designations/eu-3-15-1502